EMA orphan designation EU/3/16/1671
Active substance: 2'-O-(2-Methoxyethyl) phosphorothioate antisense oligonucleotide targeting the growth hormone receptor
Designated 2016-06-27. Orphan designation status: Withdrawn.
First published: 2016-07-26. Last updated: 2018-05-03.

Intended use: Treatment of acromegaly

Source: https://www.ema.europa.eu/en/medicines/human/orphan-designations/eu-3-16-1671